EMA orphan designation EU/3/25/3072
Active substance: Dipalmitoyl hydroxyproline
Designated 2025-06-20. Orphan designation status: Positive.
First published: 2025-07-25.

Intended use: Treatment of Netherton syndrome

Source: https://www.ema.europa.eu/en/medicines/human/orphan-designations/eu-3-25-3072